EMA orphan designation EU/3/14/1258
Active substance: Doxorubicin(6-maleimidocaproyl)hydrazone (aldoxorubicin)
Designated 2014-03-26. Orphan designation status: Withdrawn.
First published: 2014-05-07. Last updated: 2023-06-06.

Intended use: Treatment of soft tissue sarcoma

Source: https://www.ema.europa.eu/en/medicines/human/orphan-designations/eu-3-14-1258